EMA orphan designation EU/3/13/1190
Active substance: recombinant human monoclonal IgM antibody targeting glucose regulated protein 78
Designated 2013-10-07. Orphan designation status: Positive.
First published: 2013-10-31. Last updated: 2016-11-18.

Intended use: Treatment of plasma cell myeloma

Source: https://www.ema.europa.eu/en/medicines/human/orphan-designations/eu-3-13-1190